EMA orphan designation EU/3/25/3101
Active substance: adeno-associated viral vector serotype 9 containing the human CTNNB1 gene
Designated 2025-07-18. Orphan designation status: Positive.
First published: 2025-09-04.

Intended use: Treatment of autosomal dominant polycystic kidney disease

Source: https://www.ema.europa.eu/en/medicines/human/orphan-designations/eu-3-25-3101